EMA orphan designation EU/3/05/292
Active substance: H-D-Asp-D-Gln-D-Ser-D-Arg-D-Pro-D-Val-D-Gln-D-Pro-D-Phe-D-Leu-D-Asn-D-Leu-D-Thr-D-Thr-D-Pro-D-Arg-D-Lys-D-Pro-D-Arg-D-Pro-D-Pro-D-Arg-D-Arg-D-Arg-D-Gln-D-Arg-D-Arg-D-Lys-D-Lys-D-Arg-D-Gly-NH2
Designated 2005-06-16. Orphan designation status: Positive.
First published: 2009-09-21. Last updated: 2016-04-11.

Intended use: Hearing loss (acute acoustic trauma, sudden deafness and surgery induced acoustic trauma)

Source: https://www.ema.europa.eu/en/medicines/human/orphan-designations/eu-3-05-292